EMA orphan designation EU/3/22/2595
Active substance: Glofitamab
Designated 2022-04-13. Orphan designation status: Positive.
First published: 2022-07-18.

Intended use: Treatment of mantle cell lymphoma

Source: https://www.ema.europa.eu/en/medicines/human/orphan-designations/eu-3-22-2595